EMA orphan designation EU/3/17/1935
Active substance: 1,4-diamino-2,3-dicyano-1,4-bis[2-aminophenylthio] butadiene
Designated 2017-10-12. Orphan designation status: Positive.
First published: 2018-01-15. Last updated: 2018-01-15.

Intended use: Treatment of non-traumatic subarachnoid haemorrhage

Source: https://www.ema.europa.eu/en/medicines/human/orphan-designations/eu-3-17-1935